EMA orphan designation EU/3/14/1280
Active substance: autologous CD34+ cells transduced with a lentiviral vector containing the human SGSH gene
Designated 2014-06-10. Orphan designation status: Positive.
First published: 2014-07-09. Last updated: 2022-07-14.

Intended use: Treatment of mucopolysaccharidosis, type IIIA (Sanfilippo A syndrome)

Source: https://www.ema.europa.eu/en/medicines/human/orphan-designations/eu-3-14-1280